EMA orphan designation EU/3/16/1810
Active substance: 3-pentylbenzeneacetic acid sodium salt
Designated 2017-01-12. Orphan designation status: Withdrawn.
First published: 2017-07-14. Last updated: 2022-02-28.

Intended use: Treatment of Alström syndrome

Source: https://www.ema.europa.eu/en/medicines/human/orphan-designations/eu-3-16-1810